EMA orphan designation EU/3/16/1626
Active substance: acalabrutinib
Designated 2016-03-21. Orphan designation status: Positive.
First published: 2016-05-04. Last updated: 2016-05-04.

Intended use: Treatment of lymphoplasmacytic lymphoma

Source: https://www.ema.europa.eu/en/medicines/human/orphan-designations/eu-3-16-1626